EMA orphan designation EU/3/04/196
Active substance: ciclosporin
Designated 2004-04-14. Orphan designation status: Withdrawn.
First published: 2008-01-22. Last updated: 2008-01-22.

Intended use: Treatment of atopic keratoconjunctivitis

Source: https://www.ema.europa.eu/en/medicines/human/orphan-designations/eu-3-04-196